EMA orphan designation EU/3/05/280
Active substance: acadesine
Designated 2005-05-27. Orphan designation status: Positive.
First published: 2009-09-17. Last updated: 2013-07-31.

Intended use: Treatment of B-cell chronic lymphocytic leukaemia

Source: https://www.ema.europa.eu/en/medicines/human/orphan-designations/eu-3-05-280